EMA orphan designation EU/3/12/1041
Designated 2012-08-09. Orphan designation status: Positive.
First published: 2012-09-26. Last updated: 2012-09-26.

Intended use: Treatment of primary biliary cirrhosis

Source: https://www.ema.europa.eu/en/medicines/human/orphan-designations/eu-3-12-1041